EMA orphan designation EU/3/14/1298
Active substance: synthetic double-stranded siRNA oligonucleotide directed against antithrombin mRNA and covalently linked to a ligand containing three N-acetylgalactosamine residues (fitusiran)
Designated 2014-07-29. Orphan designation status: Positive.
First published: 2014-09-01. Last updated: 2023-07-18.

Intended use: Treatment of haemophilia B

Source: https://www.ema.europa.eu/en/medicines/human/orphan-designations/eu-3-14-1298